EMA orphan designation EU/3/05/339
Active substance: dasatinib
Designated 2005-12-23. Orphan designation status: Expired.
First published: 2007-02-28. Last updated: 2017-02-08.

Intended use: Treatment of chronic myeloid leukaemia

Source: https://www.ema.europa.eu/en/medicines/human/orphan-designations/eu-3-05-339